EMA orphan designation EU/3/25/3095
Active substance: luminol monosodium
Designated 2025-07-18. Orphan designation status: Positive.
First published: 2025-09-04.

Intended use: Treatment of Duchenne muscular dystrophy

Source: https://www.ema.europa.eu/en/medicines/human/orphan-designations/eu-3-25-3095